EMA orphan designation EU/3/02/129
Active substance: G17(9) gastrin-Diphtheria Toxoid Conjugate
Designated 2003-01-24. Orphan designation status: Positive.
First published: 2009-09-21. Last updated: 2009-09-21.

Intended use: Treatment of pancreatic cancer

Source: https://www.ema.europa.eu/en/medicines/human/orphan-designations/eu-3-02-129